EMA orphan designation EU/3/19/2240
Active substance: nicardipine
Designated 2020-01-09. Orphan designation status: Positive.
First published: 2020-05-19.

Intended use: Treatment of non-traumatic subarachnoid haemorrhage

Source: https://www.ema.europa.eu/en/medicines/human/orphan-designations/eu-3-19-2240